EMA orphan designation EU/3/10/727
Active substance: Lentiviral vector containing the human MYO7A gene
Designated 2010-03-23. Orphan designation status: Positive.
First published: 2010-03-26. Last updated: 2023-03-13.

Intended use: Treatment of retinitis pigmentosa in Usher syndrome 1B

Source: https://www.ema.europa.eu/en/medicines/human/orphan-designations/eu-3-10-727